EMA orphan designation EU/3/22/2625: Ekterly
Active substance: sebetralstat
Designated 2022-06-21. Orphan designation status: Positive. EMA product number: EMEA/H/C/006211.
First published: 2022-09-15.

Intended use: Treatment of hereditary angioedema

Source: https://www.ema.europa.eu/en/medicines/human/orphan-designations/eu-3-22-2625